EMA orphan designation EU/3/17/1880
Active substance: avacopan
Designated 2017-06-20. Orphan designation status: Positive.
First published: 2017-07-17. Last updated: 2019-03-06.

Intended use: Treatment of C3 glomerulopathy

Source: https://www.ema.europa.eu/en/medicines/human/orphan-designations/eu-3-17-1880